EMA orphan designation EU/3/16/1623
Active substance: adeno-associated viral vector serotype 8 encoding human ornithine transcarbamylase (avalotcagene ontaparvovec)
Designated 2016-03-21. Orphan designation status: Positive.
First published: 2016-05-04. Last updated: 2020-11-11.

Intended use: Treatment of ornithine transcarbamylase deficiency

Source: https://www.ema.europa.eu/en/medicines/human/orphan-designations/eu-3-16-1623